EMA orphan designation EU/3/20/2354
Active substance: alisitol; retinol palmitate; zinc gluconate
Designated 2020-11-13. Orphan designation status: Positive.
First published: 2021-02-15. Last updated: 2024-04-08.

Intended use: Treatment of microvillus inclusion disease

Source: https://www.ema.europa.eu/en/medicines/human/orphan-designations/eu-3-20-2354